EMA orphan designation EU/3/24/2934
Active substance: L-methionine
Designated 2024-06-28. Orphan designation status: Positive.
First published: 2024-08-22.

Intended use: Treatment of pulmonary alveolar proteinosis

Source: https://www.ema.europa.eu/en/medicines/human/orphan-designations/eu-3-24-2934